EMA orphan designation EU/3/20/2269
Active substance: tislelizumab
Orphan designation status: Withdrawn.
First published: 2024-03-15.

Intended use: Treatment of hepatocellular carcinoma

Source: https://www.ema.europa.eu/en/medicines/human/orphan-designations/eu-3-20-2269